EMA orphan designation EU/3/02/103
Active substance: recombinant human porphobilinogen deaminase
Designated 2002-06-12. Orphan designation status: Withdrawn.
First published: 2003-01-08. Last updated: 2020-08-12.

Intended use: Treatment of acute intermittent porphyria

Source: https://www.ema.europa.eu/en/medicines/human/orphan-designations/eu-3-02-103